EMA orphan designation EU/3/12/1044
Active substance: folic acid
Designated 2012-09-10. Orphan designation status: Withdrawn.
First published: 2012-09-26. Last updated: 2017-02-07.

Intended use: Diagnosis of positive folate-receptor status in ovarian cancer

Source: https://www.ema.europa.eu/en/medicines/human/orphan-designations/eu-3-12-1044